EMA orphan designation EU/3/14/1297
Active substance: synthetic double-stranded siRNA oligonucleotide directed against antithrombin mRNA and covalently linked to a ligand containing three N-acetylgalactosamine residues (fitusiran)
Designated 2014-07-29. Orphan designation status: Positive.
First published: 2014-09-01. Last updated: 2023-07-18.

Intended use: Treatment of haemophilia A

Source: https://www.ema.europa.eu/en/medicines/human/orphan-designations/eu-3-14-1297